EMA orphan designation EU/3/08/608
Active substance: yttrium (90Y)-DOTA-radiolabelled humanized monoclonal antibody against mucin 1
Designated 2009-02-06. Orphan designation status: Withdrawn.
First published: 2009-06-29. Last updated: 2020-03-06.

Intended use: Treatment of pancreatic cancer

Source: https://www.ema.europa.eu/en/medicines/human/orphan-designations/eu-3-08-608